EMA orphan designation EU/3/16/1613
Active substance: tolfenamic acid
Designated 2016-02-17. Orphan designation status: Positive.
First published: 2016-04-07. Last updated: 2016-04-07.

Intended use: Treatment of progressive supranuclear palsy

Source: https://www.ema.europa.eu/en/medicines/human/orphan-designations/eu-3-16-1613